EMA orphan designation EU/3/19/2233
Active substance: navitoclax
Designated 2019-12-16. Orphan designation status: Withdrawn.
First published: 2020-02-25. Last updated: 2026-05-26.

Intended use: Treatment of myelofibrosis

Source: https://www.ema.europa.eu/en/medicines/human/orphan-designations/eu-3-19-2233